EMA orphan designation EU/3/01/043
Active substance: human Milk Fat Globule 1; yttrium (90Y) human milk fat globule 1 - S p isothiocyanatobenzyl-diethylenetriaminepentaacetic acid
Designated 2001-05-30. Orphan designation status: Withdrawn.
First published: 2004-06-02. Last updated: 2004-06-02.

Intended use: Treatment of ovarian cancer

Source: https://www.ema.europa.eu/en/medicines/human/orphan-designations/eu-3-01-043